EMA orphan designation EU/3/17/1895
Active substance: retinol
Designated 2017-07-17. Orphan designation status: Positive.
First published: 2017-08-15. Last updated: 2017-08-15.

Intended use: Prevention of retinopathy of prematurity

Source: https://www.ema.europa.eu/en/medicines/human/orphan-designations/eu-3-17-1895